EMA orphan designation EU/3/12/1087: Ngenla
Active substance: recombinant modified human growth hormone
Designated 2013-01-24. Orphan designation status: Positive. EMA product number: EMEA/H/C/005633.
First published: 2013-03-01. Last updated: 2022-03-03.

Intended use: Treatment of growth hormone deficiency

Source: https://www.ema.europa.eu/en/medicines/human/orphan-designations/eu-3-12-1087